EMA orphan designation EU/3/15/1450: SomaKit TOC
Active substance: Gallium (68Ga)-edotreotide
Designated 2015-03-19. Orphan designation status: Positive. EMA product number: EMEA/H/C/004140.
First published: 2015-05-11. Last updated: 2023-05-03.

Intended use: Diagnosis of gastro-entero-pancreatic neuroendocrine tumours

Source: https://www.ema.europa.eu/en/medicines/human/orphan-designations/eu-3-15-1450